EMA orphan designation EU/3/21/2530
Active substance: olverembatinib
Designated 2021-11-12. Orphan designation status: Positive.
First published: 2022-06-15.

Intended use: Treatment of chronic myeloid leukaemia

Source: https://www.ema.europa.eu/en/medicines/human/orphan-designations/eu-3-21-2530